EMA orphan designation EU/3/12/1082
Active substance: triheptanoin
Designated 2012-12-06. Orphan designation status: Withdrawn.
First published: 2013-01-25. Last updated: 2016-08-11.

Intended use: Treatment of long-chain 3-hydroxyacyl-coenzyme A dehydrogenase deficiency

Source: https://www.ema.europa.eu/en/medicines/human/orphan-designations/eu-3-12-1082